EMA orphan designation EU/3/07/467
Active substance: eltrombopag olamine
Designated 2007-08-03. Orphan designation status: Withdrawn.
First published: 2009-09-21. Last updated: 2012-01-12.

Intended use: Treatment of idiopathic thrombocytopenic purpura

Source: https://www.ema.europa.eu/en/medicines/human/orphan-designations/eu-3-07-467